EMA orphan designation EU/3/13/1160
Active substance: Idelalisib
Designated 2013-07-17. Orphan designation status: Withdrawn.
First published: 2013-08-22. Last updated: 2013-11-18.

Intended use: Treatment of lymphoplasmacytic lymphoma

Source: https://www.ema.europa.eu/en/medicines/human/orphan-designations/eu-3-13-1160